EMA orphan designation EU/3/19/2244
Active substance: ziritaxestat
Designated 2020-01-09. Orphan designation status: Withdrawn.
First published: 2020-05-20. Last updated: 2021-04-06.

Intended use: Treatment of systemic sclerosis

Source: https://www.ema.europa.eu/en/medicines/human/orphan-designations/eu-3-19-2244